EMA orphan designation EU/3/17/1954
Active substance: venetoclax
Designated 2017-12-12. Orphan designation status: Withdrawn.
First published: 2018-01-23. Last updated: 2025-03-11.

Intended use: Treatment of mantle cell lymphoma

Source: https://www.ema.europa.eu/en/medicines/human/orphan-designations/eu-3-17-1954